EMA orphan designation EU/3/25/3132
Active substance: sonpiretigene isteparvovec
Designated 2025-08-22. Orphan designation status: Positive.
First published: 2026-04-10.

Intended use: Treatment of non-syndromic inherited retinal dystrophies of the cone-dominant phenotype

Source: https://www.ema.europa.eu/en/medicines/human/orphan-designations/eu-3-25-3132